EMA orphan designation EU/3/25/3182
Active substance: canvuparatide
Designated 2026-01-09. Orphan designation status: Positive.
First published: 2026-03-25.

Intended use: Treatment of hypoparathyroidism

Source: https://www.ema.europa.eu/en/medicines/human/orphan-designations/eu-3-25-3182